EMA orphan designation EU/3/05/270: Oncophage
Active substance: autologous Tumor-Derived gp96 Heat Shock Protein-Peptide Complex
Designated 2005-04-11. Orphan designation status: Positive. EMA product number: EMEA/H/C/001072.
First published: 2006-01-04. Last updated: 2006-01-04.

Intended use: Treatment of renal-cell carcinoma

Source: https://www.ema.europa.eu/en/medicines/human/orphan-designations/eu-3-05-270